EMA orphan designation EU/3/24/3013
Active substance: mRNA encoding Cas9-deaminase, single guide RNA against the human TGM1 gene
Designated 2024-12-13. Orphan designation status: Positive.
First published: 2025-01-13.

Intended use: Treatment of autosomal recessive congenital ichthyosis

Source: https://www.ema.europa.eu/en/medicines/human/orphan-designations/eu-3-24-3013